EMA orphan designation EU/3/01/048
Active substance: ziconotide
Designated 2001-07-09. Orphan designation status: Expired.
First published: 2007-07-19. Last updated: 2015-05-08.

Intended use: Treatment of chronic pain requiring intraspinal analgesia

Source: https://www.ema.europa.eu/en/medicines/human/orphan-designations/eu-3-01-048